EMA orphan designation EU/3/22/2680
Active substance: Epetraborole
Designated 2022-08-10. Orphan designation status: Positive.
First published: 2022-12-15. Last updated: 2024-10-02.

Intended use: Treatment of nontuberculous mycobacterial lung disease

Source: https://www.ema.europa.eu/en/medicines/human/orphan-designations/eu-3-22-2680